EMA orphan designation EU/3/17/1919
Active substance: bitopertin
Designated 2017-10-16. Orphan designation status: Withdrawn.
First published: 2018-01-10. Last updated: 2018-10-24.

Intended use: Treatment of beta thalassaemia intermedia and major

Source: https://www.ema.europa.eu/en/medicines/human/orphan-designations/eu-3-17-1919